EMA orphan designation EU/3/11/937
Active substance: alpha-tocotrienol quinone
Designated 2011-12-09. Orphan designation status: Withdrawn.
First published: 2011-12-20. Last updated: 2026-05-18.

Intended use: Treatment of Leigh syndrome

Source: https://www.ema.europa.eu/en/medicines/human/orphan-designations/eu-3-11-937